EMA orphan designation EU/3/07/461
Active substance: Human plasminogen
Designated 2007-08-03. Orphan designation status: Positive.
First published: 2008-01-17. Last updated: 2008-01-17.

Intended use: Treatment of ligneous conjunctivitis

Source: https://www.ema.europa.eu/en/medicines/human/orphan-designations/eu-3-07-461